EMA orphan designation EU/3/09/674
Active substance: recombinant human serum amyloid P
Designated 2009-10-09. Orphan designation status: Withdrawn.
First published: 2009-10-20. Last updated: 2014-04-03.

Intended use: Prevention of scarring post glaucoma filtration surgery

Source: https://www.ema.europa.eu/en/medicines/human/orphan-designations/eu-3-09-674